EMA orphan designation EU/3/12/1020
Active substance: recombinant human pentraxin-2
Designated 2012-07-17. Orphan designation status: Positive.
First published: 2012-08-30. Last updated: 2020-04-24.

Intended use: Treatment of idiopathic pulmonary fibrosis

Source: https://www.ema.europa.eu/en/medicines/human/orphan-designations/eu-3-12-1020